EMA orphan designation EU/3/19/2231
Active substance: H-Leu-Pro-Pro-Leu-Pro-Tyr-Pro-OH
Designated 2019-12-16. Orphan designation status: Positive.
First published: 2020-02-25.

Intended use: Treatment of amyotrophic lateral sclerosis

Source: https://www.ema.europa.eu/en/medicines/human/orphan-designations/eu-3-19-2231